EMA orphan designation EU/3/16/1813
Active substance: autologous dendritic cells incubated ex vivo with zebularine and factor VIII
Designated 2017-01-12. Orphan designation status: Positive.
First published: 2017-07-14. Last updated: 2017-07-14.

Intended use: Treatment of haemophilia A

Source: https://www.ema.europa.eu/en/medicines/human/orphan-designations/eu-3-16-1813